EMA orphan designation EU/3/23/2775
Active substance: 2-{4-[4-(4-{5-[(1S)-1-amino-1-(4-fluorophenyl) ethyl]pyrimidin-2-yl}piperazin-1-yl)pyrrolo[2,1-f] [1,2,4]triazin-6-yl]-1H-pyrazol-1-yl}ethan-1-ol
Designated 2023-04-21. Orphan designation status: Positive.
First published: 2024-01-25.

Intended use: Treatment of mastocytosis

Source: https://www.ema.europa.eu/en/medicines/human/orphan-designations/eu-3-23-2775